EMA orphan designation EU/3/14/1266: Kymriah
Active substance: autologous T cells transduced with lentiviral vector containing a chimeric antigen receptor directed against CD19 (tisagenlecleucel)
Designated 2014-04-29. Orphan designation status: Positive. EMA product number: EMEA/H/C/004090.
First published: 2014-06-04. Last updated: 2018-06-13.

Intended use: Treatment of B-lymphoblastic leukaemia/lymphoma

Source: https://www.ema.europa.eu/en/medicines/human/orphan-designations/eu-3-14-1266